EMA orphan designation EU/3/11/858
Active substance: R-baclofen
Designated 2011-04-15. Orphan designation status: Positive.
First published: 2011-04-26. Last updated: 2011-04-26.

Intended use: Treatment of fragile X syndrome

Source: https://www.ema.europa.eu/en/medicines/human/orphan-designations/eu-3-11-858